EMA orphan designation EU/3/23/2809
Active substance: chimeric human-murine IgG1 kappa monoclonal antibody against TNF alfa
Designated 2023-07-25. Orphan designation status: Positive.
First published: 2024-02-01.

Intended use: Treatment of sarcoidosis

Source: https://www.ema.europa.eu/en/medicines/human/orphan-designations/eu-3-23-2809